EMA orphan designation EU/3/00/009
Active substance: thalidomide
Designated 2000-12-29. Orphan designation status: Withdrawn.
First published: 2009-05-15. Last updated: 2009-05-15.

Intended use: Treatment of erythema nodosum leprosum (ENL) or type II lepra reactions

Source: https://www.ema.europa.eu/en/medicines/human/orphan-designations/eu-3-00-009